EMA orphan designation EU/3/24/3012
Active substance: tobevibart
Designated 2024-12-13. Orphan designation status: Positive.
First published: 2025-01-15.

Intended use: Treatment of hepatitis delta virus infection

Source: https://www.ema.europa.eu/en/medicines/human/orphan-designations/eu-3-24-3012